EMA orphan designation EU/3/14/1272
Active substance: recombinant human alpha 1 chain homotrimer of type VII collagen
Designated 2014-06-04. Orphan designation status: Positive.
First published: 2014-07-10. Last updated: 2021-10-13.

Intended use: Treatment of epidermolysis bullosa

Source: https://www.ema.europa.eu/en/medicines/human/orphan-designations/eu-3-14-1272